EMA orphan designation EU/3/09/628
Active substance: Mercaptopurine
Designated 2009-04-30. Orphan designation status: Expired.
First published: 2009-05-12. Last updated: 2022-05-05.

Intended use: Treatment of acute lymphoblastic leukaemia

Source: https://www.ema.europa.eu/en/medicines/human/orphan-designations/eu-3-09-628